EMA orphan designation EU/3/04/199
Active substance: tetrahydrobiopterin
Designated 2004-06-08. Orphan designation status: Withdrawn.
First published: 2008-08-18. Last updated: 2021-01-11.

Intended use: Treatment of hyperphenylalaninaemia

Source: https://www.ema.europa.eu/en/medicines/human/orphan-designations/eu-3-04-199